EMA orphan designation EU/3/25/3051
Active substance: sirolimus
Designated 2025-04-16. Orphan designation status: Positive.
First published: 2025-06-10.

Intended use: Treatment of familial adenomatous polyposis (FAP)

Source: https://www.ema.europa.eu/en/medicines/human/orphan-designations/eu-3-25-3051